EMA orphan designation EU/3/04/213: Bosatria
Active substance: Mepolizumab
Designated 2004-07-29. Orphan designation status: Withdrawn. EMA product number: EMEA/H/C/001069.
First published: 2009-09-23. Last updated: 2020-10-23.

Intended use: Treatment of hypereosinophilic syndrome

Source: https://www.ema.europa.eu/en/medicines/human/orphan-designations/eu-3-04-213